EMA orphan designation EU/3/25/3032
Active substance: (R)-(3-(2'-cyclopropyl-3-(hydroxymethyl)-[1,1'-biphenyl]-4-yl)pyrrolidin-1-yl)(5-hydroxy-6-methylpyridin-2-yl)methanone
Designated 2025-02-26. Orphan designation status: Positive.
First published: 2025-03-11.

Intended use: Treatment of Olmsted syndrome

Source: https://www.ema.europa.eu/en/medicines/human/orphan-designations/eu-3-25-3032